EMA orphan designation EU/3/04/200
Active substance: (2-aminoethyl) carbamic acid (2R,5S,8S,11S,14R,17S,19aS)-11-(4-aminobutyl)-5-benzyl-8-(4-benzyloxy benzyl)-14-(1H-indol-3-ylmethyl)-4,7,10,13,16,19-hexaoxo-17-phenyloctadecahydro-3a,6,9,12,15,18-hexaazacyclopentacyclooctadecen-2-yl ester, di[(S)-2-aminosu (pasireotide)
Designated 2004-06-08. Orphan designation status: Withdrawn.
First published: 2006-09-03. Last updated: 2017-08-16.

Intended use: Treatment of functional gastro-entero-pancreatic endocrine tumours

Source: https://www.ema.europa.eu/en/medicines/human/orphan-designations/eu-3-04-200